EMA orphan designation EU/3/13/1182
Active substance: cladribine
Designated 2013-08-05. Orphan designation status: Positive.
First published: 2013-09-16. Last updated: 2013-09-16.

Intended use: Treatment of mastocytosis

Source: https://www.ema.europa.eu/en/medicines/human/orphan-designations/eu-3-13-1182